EMA orphan designation EU/3/11/942: Vyxeos liposomal (previously Vyxeos)
Active substance: cytarabine; daunorubicin
Designated 2012-01-11. Orphan designation status: Positive. EMA product number: EMEA/H/C/004282.
First published: 2012-02-01. Last updated: 2014-10-08.

Intended use: Treatment of acute myeloid leukaemia

Source: https://www.ema.europa.eu/en/medicines/human/orphan-designations/eu-3-11-942